EMA orphan designation EU/3/09/692
Active substance: 16-base single-stranded PNA oligonucleotide linked to a 7-aminoacid peptide
Designated 2009-11-25. Orphan designation status: Positive.
First published: 2009-12-10. Last updated: 2014-04-01.

Intended use: Treatment of neuroblastoma

Source: https://www.ema.europa.eu/en/medicines/human/orphan-designations/eu-3-09-692